EMA orphan designation EU/3/15/1535
Active substance: fibrinogen-coated albumin spheres
Designated 2015-08-10. Orphan designation status: Positive.
First published: 2015-10-02. Last updated: 2019-05-22.

Intended use: Treatment of acute radiation syndrome

Source: https://www.ema.europa.eu/en/medicines/human/orphan-designations/eu-3-15-1535